EMA orphan designation EU/3/10/745
Active substance: dexamethasone
Designated 2010-06-09. Orphan designation status: Withdrawn.
First published: 2010-06-23. Last updated: 2018-02-12.

Intended use: Treatment of multiple myeloma

Source: https://www.ema.europa.eu/en/medicines/human/orphan-designations/eu-3-10-745